EMA orphan designation EU/3/17/1947
Active substance: 2-isopropyl-3H-naphtho[1,2-d]imidazole-4,5-dione
Designated 2017-12-12. Orphan designation status: Positive.
First published: 2018-01-23. Last updated: 2021-02-03.

Intended use: Treatment of mitochondrial encephalomyopathy, lactic acidosis and stroke-like episodes

Source: https://www.ema.europa.eu/en/medicines/human/orphan-designations/eu-3-17-1947